EMA orphan designation EU/3/19/2220: Tecartus
Active substance: autologous peripheral blood T cells CD4 and CD8 selected and CD3 and CD28 activated transduced with retroviral vector expressing anti CD19 CD28/CD3-zeta chimeric antigen receptor and cultured (brexucabtagene autoleucel)
Designated 2019-11-13. Orphan designation status: Positive. EMA product number: EMEA/H/C/005102.
First published: 2020-02-13. Last updated: 2021-09-15.

Intended use: Treatment of mantle cell lymphoma

Source: https://www.ema.europa.eu/en/medicines/human/orphan-designations/eu-3-19-2220